EMA orphan designation EU/3/04/253
Active substance: Val-Leu-Gln-Glu-Leu-Asn-Val-Thr-Val (Pr1 nanopeptide, sequence 169-177, of proteinase 3)
Designated 2004-12-21. Orphan designation status: Withdrawn.
First published: 2006-01-06. Last updated: 2009-11-19.

Intended use: Treatment of acute myeloid leukaemia

Source: https://www.ema.europa.eu/en/medicines/human/orphan-designations/eu-3-04-253